EMA orphan designation EU/3/14/1329
Active substance: variant of recombinant human fibroblast growth factor 19
Designated 2014-08-22. Orphan designation status: Withdrawn.
First published: 2014-10-02. Last updated: 2022-10-21.

Intended use: Treatment of primary biliary cirrhosis

Source: https://www.ema.europa.eu/en/medicines/human/orphan-designations/eu-3-14-1329